EMA orphan designation EU/3/10/739
Active substance: Glyceryl tri-(4-phenylbutyrate)
Designated 2010-06-10. Orphan designation status: Positive.
First published: 2010-06-24. Last updated: 2022-11-18.

Intended use: Treatment of citrullinaemia type 2

Source: https://www.ema.europa.eu/en/medicines/human/orphan-designations/eu-3-10-739